EMA orphan designation EU/3/08/546
Designated 2008-06-03. Orphan designation status: Positive.
First published: 2008-08-18. Last updated: 2013-10-17.

Intended use: Treatment of congenital alpha-1 antitrypsin deficiency

Source: https://www.ema.europa.eu/en/medicines/human/orphan-designations/eu-3-08-546